EMA orphan designation EU/3/14/1299
Active substance: riociguat
Designated 2014-07-29. Orphan designation status: Withdrawn.
First published: 2014-09-01. Last updated: 2019-02-21.

Intended use: Treatment of systemic sclerosis

Source: https://www.ema.europa.eu/en/medicines/human/orphan-designations/eu-3-14-1299